EMA orphan designation EU/3/04/235
Active substance: recombinant human insulin-like growth factor-I; recombinant human insulin-like growth factor binding protein-3
Designated 2004-10-20. Orphan designation status: Withdrawn.
First published: 2009-02-20. Last updated: 2009-02-20.

Intended use: Treatment of type B extreme insulin resistance syndrome

Source: https://www.ema.europa.eu/en/medicines/human/orphan-designations/eu-3-04-235